EMA orphan designation EU/3/14/1321
Active substance: adeno-associated viral vector serotype 8 containing the human UGT1A1 gene (volrubigene ralaparvovec)
Designated 2014-08-22. Orphan designation status: Withdrawn.
First published: 2014-10-01. Last updated: 2021-03-19.

Intended use: Treatment of Crigler-Najjar syndrome

Source: https://www.ema.europa.eu/en/medicines/human/orphan-designations/eu-3-14-1321